EMA orphan designation EU/3/06/378
Active substance: mecasermin rinfabate
Designated 2006-06-20. Orphan designation status: Withdrawn.
First published: 2009-04-24. Last updated: 2009-04-24.

Intended use: Treatment of primary insulin-like growth factor-1 deficiency due to molecular or genetic defects

Source: https://www.ema.europa.eu/en/medicines/human/orphan-designations/eu-3-06-378